EMA orphan designation EU/3/15/1443
Active substance: recombinant human glutamate oxaloacetate transaminase 1
Designated 2015-02-12. Orphan designation status: Withdrawn.
First published: 2015-04-09. Last updated: 2021-04-07.

Intended use: Treatment of glioma

Source: https://www.ema.europa.eu/en/medicines/human/orphan-designations/eu-3-15-1443